EMA orphan designation EU/3/16/1670
Active substance: vemurafenib
Designated 2016-05-30. Orphan designation status: Positive.
First published: 2016-06-24. Last updated: 2016-06-24.

Intended use: Treatment of Langerhans' cell histiocytosis

Source: https://www.ema.europa.eu/en/medicines/human/orphan-designations/eu-3-16-1670